EMA orphan designation EU/3/14/1332
Active substance: Macromolecular conjugate of heparin sodium on a polymer backbone
Designated 2014-08-22. Orphan designation status: Positive.
First published: 2014-10-02. Last updated: 2016-03-29.

Intended use: Prevention of ischaemia/reperfusion injury associated with solid organ transplantation

Source: https://www.ema.europa.eu/en/medicines/human/orphan-designations/eu-3-14-1332